EMA orphan designation EU/3/07/504
Active substance: irinotecan hydrochloride
Designated 2007-11-30. Orphan designation status: Withdrawn.
First published: 2008-07-02. Last updated: 2017-04-25.

Intended use: Treatment of glioma

Source: https://www.ema.europa.eu/en/medicines/human/orphan-designations/eu-3-07-504